EMA orphan designation EU/3/15/1479
Active substance: recombinant monoclonal IgG1 antibody against T-cell immune response cDNA 7
Designated 2015-04-24. Orphan designation status: Positive.
First published: 2015-05-22. Last updated: 2015-05-22.

Intended use: Prevention of graft rejection following solid organ transplantation

Source: https://www.ema.europa.eu/en/medicines/human/orphan-designations/eu-3-15-1479